EMA orphan designation EU/3/06/418
Active substance: Iodine (131I) anti-tenascin monoclonal antibody 81C6
Designated 2006-10-30. Orphan designation status: Withdrawn.
First published: 2009-06-15. Last updated: 2016-02-10.

Intended use: Treatment of glioma

Source: https://www.ema.europa.eu/en/medicines/human/orphan-designations/eu-3-06-418